EMA orphan designation EU/3/11/900
Active substance: 20-pentaerythritol poly (oxy-1,2-ethanediyl)-carboxymethyl-glycinate-7-ethyl-10-hydroxycamptothecine 10-[1,4'-bipiperidine]-1'-carboxylate
Designated 2011-09-27. Orphan designation status: Positive.
First published: 2011-10-17. Last updated: 2011-10-17.

Intended use: Treatment of ovarian cancer

Source: https://www.ema.europa.eu/en/medicines/human/orphan-designations/eu-3-11-900